EMA orphan designation EU/3/25/3047
Active substance: Fragment antibody targeting human TfR1 conjugated to phosphorodiamidate morpholino oligomer
Designated 2025-04-16. Orphan designation status: Positive.
First published: 2025-06-10.

Intended use: Treatment of Duchenne muscular dystrophy

Source: https://www.ema.europa.eu/en/medicines/human/orphan-designations/eu-3-25-3047